EMA orphan designation EU/3/16/1683
Active substance: melatonin
Designated 2016-06-27. Orphan designation status: Positive.
First published: 2016-07-26. Last updated: 2018-10-23.

Intended use: Treatment of neonatal sepsis

Source: https://www.ema.europa.eu/en/medicines/human/orphan-designations/eu-3-16-1683